EMA orphan designation EU/3/25/3034
Active substance: autologous CD34+ cell enriched population containing haematopoietic stem and progenitor cells transduced ex vivo with a lentiviral vector encoding the human ADA2 gene
Designated 2025-02-27. Orphan designation status: Positive.
First published: 2025-03-11.

Intended use: Treatment of adenosine deaminase 2 deficiency (DADA2)

Source: https://www.ema.europa.eu/en/medicines/human/orphan-designations/eu-3-25-3034